EMA orphan designation EU/3/14/1339: Epidyolex
Active substance: cannabidiol
Designated 2014-10-15. Orphan designation status: Positive. EMA product number: EMEA/H/C/004675.
First published: 2014-11-12. Last updated: 2023-06-14.

Intended use: Treatment of Dravet syndrome

Source: https://www.ema.europa.eu/en/medicines/human/orphan-designations/eu-3-14-1339